EMA orphan designation EU/3/18/2009
Active substance: autologous dendritic cells pulsed with killed ovarian cancer cells and matured by TLR3 ligand ex vivo
Designated 2018-04-16. Orphan designation status: Withdrawn.
First published: 2018-05-17. Last updated: 2022-08-17.

Intended use: Treatment of ovarian cancer

Source: https://www.ema.europa.eu/en/medicines/human/orphan-designations/eu-3-18-2009